EMA orphan designation EU/3/23/2855
Active substance: 5-bromo-N-(prop-2-yn-1-yl)-2-(1H-1,2,4-triazol-1-yl)pyrimidine-4,6-diamine
Designated 2023-11-08. Orphan designation status: Positive.
First published: 2024-02-01.

Intended use: Treatment of Prader-Willi syndrome

Source: https://www.ema.europa.eu/en/medicines/human/orphan-designations/eu-3-23-2855